EMA orphan designation EU/3/05/327
Active substance: oligonucleotide phosphorothioate (TAAACGTTATAACGTTATGACGTCAT), sodium salt
Designated 2005-10-28. Orphan designation status: Positive.
First published: 2006-01-04. Last updated: 2006-01-04.

Intended use: Treatment of glioma

Source: https://www.ema.europa.eu/en/medicines/human/orphan-designations/eu-3-05-327